EMA orphan designation EU/3/22/2631
Active substance: sodium (4Z,7Z,10R,11E,13E,15Z,17S,19Z)10,17-dihydroxy-docosa-4,7,11,13,15,19-hexaenoate
Designated 2022-06-21. Orphan designation status: Positive.
First published: 2022-09-15.

Intended use: Prevention of retinopathy of prematurity

Source: https://www.ema.europa.eu/en/medicines/human/orphan-designations/eu-3-22-2631